EMA orphan designation EU/3/14/1383
Active substance: single-chain urokinase plasminogen activator
Designated 2014-12-16. Orphan designation status: Positive.
First published: 2015-02-26. Last updated: 2021-09-27.

Intended use: Treatment of pleural empyema

Source: https://www.ema.europa.eu/en/medicines/human/orphan-designations/eu-3-14-1383